EMA orphan designation EU/3/24/2991
Active substance: coramitug
Designated 2024-11-11. Orphan designation status: Positive.
First published: 2024-11-19.

Intended use: Treatment of ATTR amyloidosis

Source: https://www.ema.europa.eu/en/medicines/human/orphan-designations/eu-3-24-2991